EMA orphan designation EU/3/19/2239
Active substance: tripotassium citrate monohydrate; potassium hydrogen carbonate
Designated 2020-01-09. Orphan designation status: Positive.
First published: 2020-05-19. Last updated: 2023-04-13.

Intended use: Treatment of cystinuria

Source: https://www.ema.europa.eu/en/medicines/human/orphan-designations/eu-3-19-2239